EMA orphan designation EU/3/08/610
Active substance: Cyclopropane-1,1-dicarboxylic acid [4-(6,7-dimethoxy-quinolin-4-yloxy)-phenyl]-amide (4-fluoro-phenyl)-amide, (L)-malate salt (cabozantinib)
Designated 2009-02-06. Orphan designation status: Positive.
First published: 2009-06-29. Last updated: 2024-04-02.

Intended use: Treatment of medullary thyroid carcinoma

Source: https://www.ema.europa.eu/en/medicines/human/orphan-designations/eu-3-08-610